EMA orphan designation EU/3/15/1474
Active substance: Ecothiopate iodide
Designated 2015-04-24. Orphan designation status: Withdrawn.
First published: 2015-05-21. Last updated: 2020-12-10.

Intended use: Treatment of Stargardt's disease

Source: https://www.ema.europa.eu/en/medicines/human/orphan-designations/eu-3-15-1474